EMA orphan designation EU/3/16/1769: Oxbryta
Active substance: 2-hydroxy-6-((2-(1-isopropyl-1H-pyrazol-5-yl)pyridin-3-yl)methoxy)benzaldehyde (voxelotor)
Designated 2016-11-18. Orphan designation status: Positive. EMA product number: EMEA/H/C/004869.
First published: 2016-12-14. Last updated: 2023-05-04.

Intended use: Treatment of sickle cell disease

Source: https://www.ema.europa.eu/en/medicines/human/orphan-designations/eu-3-16-1769